EMA orphan designation EU/3/19/2238
Active substance: adeno-associated virus serotype 9 vector containing human n-acetylgalactosamine-6-sulfate sulfatase gene
Designated 2020-01-09. Orphan designation status: Positive.
First published: 2020-05-19. Last updated: 2022-07-08.

Intended use: Treatment of mucopolysaccharidosis type IVA (Morquio A Syndrome)

Source: https://www.ema.europa.eu/en/medicines/human/orphan-designations/eu-3-19-2238